EMA orphan designation EU/3/23/2793
Active substance: exenatide
Designated 2023-06-20. Orphan designation status: Positive.
First published: 2024-02-02.

Intended use: Treatment of moderate and severe closed traumatic brain injury

Source: https://www.ema.europa.eu/en/medicines/human/orphan-designations/eu-3-23-2793